EMA orphan designation EU/3/16/1771
Active substance: adeno-associated viral vector serotype 8 containing the human glucose-6-phosphatase gene (pariglasgene brecaparvovec)
Designated 2016-11-18. Orphan designation status: Positive.
First published: 2016-12-14. Last updated: 2020-11-11.

Intended use: treatment of glycogen storage disease type Ia

Source: https://www.ema.europa.eu/en/medicines/human/orphan-designations/eu-3-16-1771